EMA orphan designation EU/3/07/479
Active substance: N-adamantanyl-N'-Geranyl-ethylenediamine
Designated 2007-09-14. Orphan designation status: Positive.
First published: 2008-07-29. Last updated: 2008-07-29.

Intended use: Treatment of tuberculosis

Source: https://www.ema.europa.eu/en/medicines/human/orphan-designations/eu-3-07-479